EMA orphan designation EU/3/21/2524
Active substance: rebastinib
Designated 2021-11-12. Orphan designation status: Positive.
First published: 2022-06-09. Last updated: 2023-02-07.

Intended use: Treatment of ovarian cancer

Source: https://www.ema.europa.eu/en/medicines/human/orphan-designations/eu-3-21-2524